EMA orphan designation EU/3/16/1615
Active substance: 2-Ethylbutyl (2S)-2-{[(S)-{[(2R,3S,4R,5R)-5-(4-aminopyrrolo[2,1-f][1,2,4]triazin-7-yl)-5-cyano-3,4-dihydroxytetrahydrofuran-2-yl]methoxy}(phenoxy)phosphoryl]amino}propanoate (remdesivir)
Designated 2016-02-17. Orphan designation status: Positive.
First published: 2018-04-17. Last updated: 2018-06-13.

Intended use: Treatment of Ebola virus disease

Source: https://www.ema.europa.eu/en/medicines/human/orphan-designations/eu-3-16-1615